EMA orphan designation EU/3/04/207
Active substance: sorafenib tosilate
Designated 2004-07-29. Orphan designation status: Expired.
First published: 2009-09-23. Last updated: 2016-08-10.

Intended use: Treatment of renal-cell carcinoma

Source: https://www.ema.europa.eu/en/medicines/human/orphan-designations/eu-3-04-207